EMA orphan designation EU/3/06/362
Active substance: human heterologous liver cells
Designated 2006-04-11. Orphan designation status: Positive.
First published: 2009-04-24. Last updated: 2016-08-15.

Intended use: Treatment of acute liver failure

Source: https://www.ema.europa.eu/en/medicines/human/orphan-designations/eu-3-06-362